EMA orphan designation EU/3/15/1591
Active substance: synthetic peptide L-cysteine, L-cysteinylglycyl-L-glutaminyl-L-arginyl-L-.alpha.-glutamyl-L-threonyl-L-prolyl-L-.alpha.-glutamylglycyl-L-alanyl-L-.alpha.-glutamyl-L-alanyl-L-lysyl-L-prolyl-L-tryptophyl-L-tyrosyl-, cyclic (1.fwdarw.17)-disulfide
Designated 2015-12-14. Orphan designation status: Positive.
First published: 2016-02-03. Last updated: 2016-02-03.

Intended use: Treatment of pseudohypoaldosteronism 1B

Source: https://www.ema.europa.eu/en/medicines/human/orphan-designations/eu-3-15-1591